EMA orphan designation EU/3/05/321
Active substance: (1R, 2R, 4S)-4-{(2R)-2-[(3S,6R,7E,9R,10R,12R,14S,15E,17E,19E,21S,23S,26R, 27R,34aS)-9,27-dihydroxy-10,21-dimethoxy-6,8,12,14,20,26-hexamethyl-1,5,11,28,29-pentaoxo-1,4,5,6,9,10,11,12,13,14,21,22,23,24,25,26,27,28,29,31,32,33,34,34a-tetra-cosahydro-3H-23,2
Designated 2005-10-28. Orphan designation status: Withdrawn.
First published: 2009-09-17. Last updated: 2013-03-11.

Intended use: Treatment of primary malignant bone tumours

Source: https://www.ema.europa.eu/en/medicines/human/orphan-designations/eu-3-05-321